EMA orphan designation EU/3/03/162
Active substance: yttrium (90Y) antiferritin polyclonal antibodies
Designated 2003-10-02. Orphan designation status: Positive.
First published: 2007-05-30. Last updated: 2013-06-20.

Intended use: Treatment of Hodgkin's lymphoma

Source: https://www.ema.europa.eu/en/medicines/human/orphan-designations/eu-3-03-162